EMA orphan designation EU/3/18/2051
Active substance: synthetic antisense oligonucleotide directed against human dystrophin pre-mRNA
Designated 2018-07-31. Orphan designation status: Positive.
First published: 2018-10-10.

Intended use: Treatment of Duchenne muscular dystrophy

Source: https://www.ema.europa.eu/en/medicines/human/orphan-designations/eu-3-18-2051